EMA orphan designation EU/3/21/2533
Active substance: macitentan
Designated 2021-12-10. Orphan designation status: Withdrawn.
First published: 2022-04-13. Last updated: 2026-05-26.

Intended use: Treatment of chronic thromboembolic pulmonary hypertension

Source: https://www.ema.europa.eu/en/medicines/human/orphan-designations/eu-3-21-2533